EMA orphan designation EU/3/18/1981
Active substance: Mertansine functionalised gold nanoconjugate
Designated 2018-02-22. Orphan designation status: Withdrawn.
First published: 2018-04-11. Last updated: 2020-05-20.

Intended use: Treatment of hepatocellular carcinoma

Source: https://www.ema.europa.eu/en/medicines/human/orphan-designations/eu-3-18-1981